EMA orphan designation EU/3/12/951
Active substance: sodium phenylbutyrate
Designated 2012-02-09. Orphan designation status: Withdrawn.
First published: 2012-03-01. Last updated: 2013-08-08.

Intended use: Treatment of carbamoyl-phosphate synthase-1 deficiency

Source: https://www.ema.europa.eu/en/medicines/human/orphan-designations/eu-3-12-951